EMA orphan designation EU/3/16/1687
Active substance: recombinant protein derived from the saliva of the Ornithodoros moubata tick (nomacopan)
Designated 2016-06-27. Orphan designation status: Positive.
First published: 2016-07-26. Last updated: 2022-01-26.

Intended use: Treatment of Guillain-Barré syndrome

Source: https://www.ema.europa.eu/en/medicines/human/orphan-designations/eu-3-16-1687